EMA orphan designation EU/3/17/1836: Fintepla
Active substance: fenfluramine hydrochloride
Designated 2017-02-27. Orphan designation status: Positive. EMA product number: EMEA/H/C/003933.
First published: 2017-03-31. Last updated: 2023-04-25.

Intended use: Treatment of Lennox-Gastaut syndrome

Source: https://www.ema.europa.eu/en/medicines/human/orphan-designations/eu-3-17-1836